EMA orphan designation EU/3/17/1950
Active substance: adenovirus associated viral vector serotype 8 containing the human AIPL1 gene
Designated 2017-12-12. Orphan designation status: Positive.
First published: 2018-01-24. Last updated: 2024-10-22.

Intended use: Treatment of Leber's congenital amaurosis

Source: https://www.ema.europa.eu/en/medicines/human/orphan-designations/eu-3-17-1950